EMA orphan designation EU/3/09/657
Active substance: recombinant human N-acetylgalactosamine-6-sulfatase (elosulfase alfa)
Designated 2009-07-24. Orphan designation status: Withdrawn.
First published: 2009-09-11. Last updated: 2025-10-23.

Intended use: Treatment of mucopolysaccharidosis, type IVA (Morquio A syndrome)

Source: https://www.ema.europa.eu/en/medicines/human/orphan-designations/eu-3-09-657